EMA orphan designation EU/3/16/1618
Active substance: N-(4-Methoxyphenyl)-N,2,6-trimethylfuro[2,3-d]pyrimidin-4-amine
Designated 2016-02-16. Orphan designation status: Positive.
First published: 2016-04-07. Last updated: 2016-04-07.

Intended use: Treatment of glioma

Source: https://www.ema.europa.eu/en/medicines/human/orphan-designations/eu-3-16-1618